EMA orphan designation EU/3/23/2800
Active substance: cannabidiol
Designated 2023-07-25. Orphan designation status: Positive.
First published: 2024-01-30.

Intended use: Treatment of Leigh syndrome

Source: https://www.ema.europa.eu/en/medicines/human/orphan-designations/eu-3-23-2800